EMA orphan designation EU/3/09/724
Active substance: recombinant human monoclonal antibody to human interleukin (IL)-17A of the IgG1/k class
Designated 2010-02-02. Orphan designation status: Withdrawn.
First published: 2010-03-04. Last updated: 2015-03-06.

Intended use: Treatment of chronic non-infectious uveitis

Source: https://www.ema.europa.eu/en/medicines/human/orphan-designations/eu-3-09-724